EMA orphan designation EU/3/19/2196
Active substance: recombinant self-complementary adeno-associated viral vector serotype 9 containing the human CLN3 gene
Designated 2019-08-21. Orphan designation status: Positive.
First published: 2020-01-16. Last updated: 2024-10-22.

Intended use: Treatment of neuronal ceroid lipofuscinosis

Source: https://www.ema.europa.eu/en/medicines/human/orphan-designations/eu-3-19-2196